EMA orphan designation EU/3/15/1498
Active substance: 2-(7-ethoxy-4-(3-fluorophenyl)-1-oxophthalazin-2(1H)-yl)-N-methyl-N-(2-methylbenzo[d]oxazol-6-yl) acetamide
Designated 2015-05-21. Orphan designation status: Withdrawn.
First published: 2015-06-30. Last updated: 2020-04-16.

Intended use: Treatment of cystic fibrosis

Source: https://www.ema.europa.eu/en/medicines/human/orphan-designations/eu-3-15-1498